EMA orphan designation EU/3/10/749: Gamifant
Active substance: recombinant human anti-interferon gamma monoclonal antibody (emapalumab)
Designated 2010-06-09. Orphan designation status: Positive. EMA product number: EMEA/H/C/004386.
First published: 2010-06-23. Last updated: 2019-06-19.

Intended use: Treatment of haemophagocytic lymphohistiocytosis

Source: https://www.ema.europa.eu/en/medicines/human/orphan-designations/eu-3-10-749